EMA orphan designation EU/3/15/1545
Active substance: CD33-directed antibody-drug conjugate consisting of an antibody conjugated to a DNA cross-linking pyrrolobenzodiazepine dimer drug (vadastuximab talirine)
Designated 2015-08-10. Orphan designation status: Withdrawn.
First published: 2015-10-02. Last updated: 2019-07-09.

Intended use: Treatment of acute myeloid leukaemia

Source: https://www.ema.europa.eu/en/medicines/human/orphan-designations/eu-3-15-1545